EMA orphan designation EU/3/21/2418
Active substance: Ilixadencel
Designated 2021-03-26. Orphan designation status: Positive.
First published: 2022-01-19. Last updated: 2022-09-28.

Intended use: Treatment of gastrointestinal stromal tumours

Source: https://www.ema.europa.eu/en/medicines/human/orphan-designations/eu-3-21-2418